EMA orphan designation EU/3/14/1396
Active substance: adenovirus serotype 5 containing partial E1A deletion and an integrin-binding domain (tasadenoturev)
Designated 2014-12-16. Orphan designation status: Positive.
First published: 2015-02-26. Last updated: 2021-09-16.

Intended use: Treatment of glioma

Source: https://www.ema.europa.eu/en/medicines/human/orphan-designations/eu-3-14-1396